EMA orphan designation EU/3/02/109
Active substance: oregovomab
Designated 2002-07-30. Orphan designation status: Withdrawn.
First published: 2007-05-30. Last updated: 2026-05-26.

Intended use: Treatment of ovarian cancer

Source: https://www.ema.europa.eu/en/medicines/human/orphan-designations/eu-3-02-109